EMA orphan designation EU/3/20/2393
Active substance: Cyclo-L-glycyl-L-2-allylproline
Designated 2021-01-06. Orphan designation status: Positive.
First published: 2021-05-18. Last updated: 2024-10-22.

Intended use: Treatment of Pitt-Hopkins syndrome

Source: https://www.ema.europa.eu/en/medicines/human/orphan-designations/eu-3-20-2393